EMA orphan designation EU/3/09/684: Masican; Masiviera
Active substance: Masitinib mesilate
Designated 2009-10-28. Orphan designation status: Positive. EMA product number: EMEA/H/C/002670; EMEA/H/C/002659.
First published: 2009-11-18. Last updated: 2009-11-18.

Intended use: Treatment of pancreatic cancer

Source: https://www.ema.europa.eu/en/medicines/human/orphan-designations/eu-3-09-684